EMA orphan designation EU/3/14/1311
Active substance: Gevokizumab
Designated 2014-08-22. Orphan designation status: Withdrawn.
First published: 2014-09-30. Last updated: 2018-07-18.

Intended use: Treatment of Schnitzler syndrome

Source: https://www.ema.europa.eu/en/medicines/human/orphan-designations/eu-3-14-1311